EMA orphan designation EU/3/18/2002
Active substance: ribavirin
Designated 2018-03-21. Orphan designation status: Positive.
First published: 2018-05-15. Last updated: 2021-04-12.

Intended use: Treatment of Crimean-Congo haemorrhagic fever

Source: https://www.ema.europa.eu/en/medicines/human/orphan-designations/eu-3-18-2002